EMA orphan designation EU/3/25/3076
Active substance: 4-Amino-1-[(2R,4S,5S)-5-(1,2-dihydroxyethyl)-4-hydroxyoxolan-2-yl]pyrimidin-2-one
Designated 2025-06-20. Orphan designation status: Positive.
First published: 2025-07-25.

Intended use: Treatment of glioma

Source: https://www.ema.europa.eu/en/medicines/human/orphan-designations/eu-3-25-3076